EMA orphan designation EU/3/17/1914
Active substance: S)-3-((S)-2-(2-((2,6-difluorophenyl)amino)-2-oxoacetamido)propanamido)-4-oxo-5-(2,3,5,6-tetrafluorophenoxy)pentanoic acid
Designated 2017-10-16. Orphan designation status: Withdrawn.
First published: 2018-01-08. Last updated: 2026-05-26.

Intended use: Treatment of primary sclerosing cholangitis

Source: https://www.ema.europa.eu/en/medicines/human/orphan-designations/eu-3-17-1914